EMA orphan designation EU/3/24/2926
Active substance: afamelanotide
Designated 2024-05-24. Orphan designation status: Positive.
First published: 2024-08-22.

Intended use: Treatment of xeroderma pigmentosum

Source: https://www.ema.europa.eu/en/medicines/human/orphan-designations/eu-3-24-2926